EMA orphan designation EU/3/22/2715
Active substance: rovatirelin
Designated 2022-11-11. Orphan designation status: Positive.
First published: 2023-01-23. Last updated: 2024-04-08.

Intended use: Treatment of spinocerebellar ataxia

Source: https://www.ema.europa.eu/en/medicines/human/orphan-designations/eu-3-22-2715